EMA orphan designation EU/3/22/2728
Active substance: utreloxastat
Designated 2022-12-09. Orphan designation status: Withdrawn.
First published: 2023-03-13. Last updated: 2025-07-17.

Intended use: Treatment of amyotrophic lateral sclerosis

Source: https://www.ema.europa.eu/en/medicines/human/orphan-designations/eu-3-22-2728